EMA orphan designation EU/3/18/2091
Active substance: Glucagon
Designated 2018-11-19. Orphan designation status: Positive.
First published: 2019-02-19. Last updated: 2023-06-09.

Intended use: Treatment of noninsulinoma pancreatogenous hypoglycaemia syndrome

Source: https://www.ema.europa.eu/en/medicines/human/orphan-designations/eu-3-18-2091